EMA orphan designation EU/3/25/3152
Active substance: 2'-O-(2-Methoxyethyl) modified antisense oligonucleotide against MECP2 pre-mRNA
Designated 2025-11-21. Orphan designation status: Positive.
First published: 2026-04-10.

Intended use: Treatment of MECP2 duplication syndrome

Source: https://www.ema.europa.eu/en/medicines/human/orphan-designations/eu-3-25-3152